EMA orphan designation EU/3/18/2041
Active substance: 2ʹ-O-(2-methoxyethyl) antisense oligonucleotide targeting microtubule-associated protein tau pre-mRNA
Designated 2018-07-31. Orphan designation status: Positive.
First published: 2018-10-11. Last updated: 2020-10-13.

Intended use: treatment of behavioural variant frontotemporal dementia

Source: https://www.ema.europa.eu/en/medicines/human/orphan-designations/eu-3-18-2041